EMA orphan designation EU/3/24/3003
Active substance: allogeneic cardiosphere-derived cells
Designated 2024-12-13. Orphan designation status: Positive.
First published: 2025-01-15.

Intended use: Treatment of Duchenne muscular dystrophy

Source: https://www.ema.europa.eu/en/medicines/human/orphan-designations/eu-3-24-3003